EMA orphan designation EU/3/04/234
Active substance: sitaxentan sodium
Designated 2004-10-21. Orphan designation status: Withdrawn.
First published: 2007-02-28. Last updated: 2016-09-30.

Intended use: Treatment of pulmonary arterial hypertension and chronic thromboembolic pulmonary hypertension

Source: https://www.ema.europa.eu/en/medicines/human/orphan-designations/eu-3-04-234